EMA orphan designation EU/3/14/1410
Active substance: recombinant human aspartylglucosaminidase
Designated 2015-01-15. Orphan designation status: Withdrawn.
First published: 2015-03-05. Last updated: 2021-06-25.

Intended use: Treatment of aspartylglucosaminuria

Source: https://www.ema.europa.eu/en/medicines/human/orphan-designations/eu-3-14-1410